EMA orphan designation EU/3/25/3187
Active substance: 2-[[(4-Methoxy-3,5-dimethyl-2-pyridinyl)methyl]sulfinyl]-1H-benzimidazol-5-ol
Designated 2026-01-09. Orphan designation status: Positive.
First published: 2026-04-10.

Intended use: Treatment of multiple system atrophy

Source: https://www.ema.europa.eu/en/medicines/human/orphan-designations/eu-3-25-3187